EMA orphan designation EU/3/26/3217
Active substance: irpagratinib tosilate monohydrate
Designated 2026-03-25. Orphan designation status: Positive.
First published: 2026-04-22.

Intended use: Treatment of hepatocellular carcinoma

Source: https://www.ema.europa.eu/en/medicines/human/orphan-designations/eu-3-26-3217